EMA orphan designation EU/3/16/1796
Active substance: adeno-associated viral vector serotype 8 encoding engineered rhodopsin DNA-binding repressor and human rhodopsin expression cassettes
Designated 2016-12-12. Orphan designation status: Positive.
First published: 2017-01-09. Last updated: 2022-11-07.

Intended use: Treatment of retinitis pigmentosa

Source: https://www.ema.europa.eu/en/medicines/human/orphan-designations/eu-3-16-1796